EMA orphan designation EU/3/07/487
Active substance: 4-Ethoxy-2-(piperazin-1-yl)-7-(pyridin-4-yl)-5H-pyrimido[5,4-b]indol
Designated 2007-11-14. Orphan designation status: Positive.
First published: 2008-07-29. Last updated: 2008-07-29.

Intended use: Treatment of chronic lymphocytic leukaemia

Source: https://www.ema.europa.eu/en/medicines/human/orphan-designations/eu-3-07-487